EMA orphan designation EU/3/11/864
Active substance: adeno-associated viral vector containing the human ARSB gene
Designated 2011-05-13. Orphan designation status: Positive.
First published: 2011-05-27. Last updated: 2022-11-07.

Intended use: Treatment of mucopolysaccharidosis VI (Maroteaux-Lamy syndrome)

Source: https://www.ema.europa.eu/en/medicines/human/orphan-designations/eu-3-11-864